EMA orphan designation EU/3/24/3010
Active substance: elebsiran
Designated 2024-12-13. Orphan designation status: Positive.
First published: 2025-01-13.

Intended use: Treatment of hepatitis delta virus infection

Source: https://www.ema.europa.eu/en/medicines/human/orphan-designations/eu-3-24-3010